EMA orphan designation EU/3/14/1401
Active substance: riluzole
Designated 2014-12-16. Orphan designation status: Positive.
First published: 2015-02-27. Last updated: 2015-02-27.

Intended use: Treatment of traumatic spinal cord injury

Source: https://www.ema.europa.eu/en/medicines/human/orphan-designations/eu-3-14-1401